EMA orphan designation EU/3/18/1979
Active substance: human monoclonal IgG2 antibody against tissue factor pathway inhibitor
Designated 2018-02-22. Orphan designation status: Withdrawn.
First published: 2018-04-11. Last updated: 2020-03-26.

Intended use: Treatment of haemophilia A

Source: https://www.ema.europa.eu/en/medicines/human/orphan-designations/eu-3-18-1979